EMA orphan designation EU/3/12/1012
Active substance: 2S, 4R ketoconazole
Designated 2012-07-04. Orphan designation status: Positive.
First published: 2012-07-24. Last updated: 2024-10-11.

Intended use: Treatment of Cushing's syndrome

Source: https://www.ema.europa.eu/en/medicines/human/orphan-designations/eu-3-12-1012